EMA orphan designation EU/3/07/516
Active substance: recombinant human histone H1.3 and recombinant human N-bis-met-histone H1.3
Designated 2007-12-20. Orphan designation status: Positive.
First published: 2008-07-10. Last updated: 2013-03-13.

Intended use: Treatment of acute myeloid leukaemia

Source: https://www.ema.europa.eu/en/medicines/human/orphan-designations/eu-3-07-516